EMA orphan designation EU/3/19/2221
Active substance: camsirubicin
Designated 2019-11-13. Orphan designation status: Positive.
First published: 2020-02-13.

Intended use: Treatment of soft tissue sarcoma

Source: https://www.ema.europa.eu/en/medicines/human/orphan-designations/eu-3-19-2221